EMA orphan designation EU/3/14/1388
Active substance: allogeneic bone marrow derived mesenchymal cells expanded ex vivo in synthetic media
Designated 2014-12-16. Orphan designation status: Positive.
First published: 2015-02-26. Last updated: 2015-02-26.

Intended use: Prevention of graft-versus-host disease

Source: https://www.ema.europa.eu/en/medicines/human/orphan-designations/eu-3-14-1388